EMA orphan designation EU/3/18/2029
Active substance: 2-[(2S)-2-methyl-1,4-dioxa-8-azaspiro[4.5]dec-8-yl]-8-nitro-6-trifluoromethyl-4H-1,3-benzothiazin-4-one
Designated 2018-06-27. Orphan designation status: Positive.
First published: 2018-08-21. Last updated: 2018-08-21.

Intended use: Treatment of tuberculosis

Source: https://www.ema.europa.eu/en/medicines/human/orphan-designations/eu-3-18-2029